EMA orphan designation EU/3/17/1881
Active substance: decitabine; tetrahydrouridine
Designated 2017-06-20. Orphan designation status: Positive.
First published: 2017-07-17. Last updated: 2017-07-17.

Intended use: Treatment of sickle cell disease

Source: https://www.ema.europa.eu/en/medicines/human/orphan-designations/eu-3-17-1881